EMA orphan designation EU/3/23/2837
Active substance: adeno-associated virus vector serotype 9/rh74 containing the human CAPN3 gene and a target sequence of cardiac-specific microRNA
Designated 2023-10-13. Orphan designation status: Positive.
First published: 2024-01-24.

Intended use: Treatment of limb-girdle muscular dystrophy

Source: https://www.ema.europa.eu/en/medicines/human/orphan-designations/eu-3-23-2837